EMA orphan designation EU/3/09/620
Active substance: (R)-3-(4-(7H-pyrrolo[2,3-d]pyrimidin-4-yl)-1H-pyrazol-1-yl)-3-cyclopentylpropanenitrile phosphate
Designated 2009-04-03. Orphan designation status: Withdrawn.
First published: 2009-04-28. Last updated: 2015-05-08.

Intended use: Treatment of myelofibrosis secondary to polycythaemia vera or essential thrombocythaemia

Source: https://www.ema.europa.eu/en/medicines/human/orphan-designations/eu-3-09-620